EMA orphan designation EU/3/05/342
Active substance: Denufosol tetrasodium
Designated 2005-12-23. Orphan designation status: Withdrawn.
First published: 2007-07-04. Last updated: 2016-10-21.

Intended use: Treatment of cystic fibrosis

Source: https://www.ema.europa.eu/en/medicines/human/orphan-designations/eu-3-05-342